EMA orphan designation EU/3/01/056: Xenpozyme
Active substance: recombinant human acid sphingomyelinase (also known as olipudase alfa)
Orphan designation status: Positive. EMA product number: EMEA/H/C/004850.
First published: 2009-06-15. Last updated: 2023-07-18.

Intended use: Treatment of Niemann-Pick disease

Source: https://www.ema.europa.eu/en/medicines/human/orphan-designations/eu-3-01-056